EMA orphan designation EU/3/17/1970
Active substance: sirolimus
Designated 2018-01-17. Orphan designation status: Positive.
First published: 2018-03-14. Last updated: 2020-06-08.

Intended use: Treatment of sickle cell disease

Source: https://www.ema.europa.eu/en/medicines/human/orphan-designations/eu-3-17-1970